EMA orphan designation EU/3/18/2022
Active substance: Glucagon analogue linked to a human immunoglobulin Fc fragment
Designated 2018-05-25. Orphan designation status: Positive.
First published: 2018-07-24. Last updated: 2019-05-23.

Intended use: Treatment of congenital hyperinsulinism

Source: https://www.ema.europa.eu/en/medicines/human/orphan-designations/eu-3-18-2022